EMA orphan designation EU/3/02/089
Active substance: GM-CSF receptor antagonist
Designated 2002-03-18. Orphan designation status: Withdrawn.
First published: 2005-11-29. Last updated: 2005-11-29.

Intended use: Treatment of juvenile myelomonocytic leukaemia

Source: https://www.ema.europa.eu/en/medicines/human/orphan-designations/eu-3-02-089